EMA orphan designation EU/3/23/2784
Active substance: Humanised IgG4 monoclonal antibody against active complement component 1, subcomponent s
Designated 2023-05-22. Orphan designation status: Positive.
First published: 2024-01-24.

Intended use: Treatment of chronic inflammatory demyelinating polyneuropathy

Source: https://www.ema.europa.eu/en/medicines/human/orphan-designations/eu-3-23-2784